EMA orphan designation EU/3/07/466
Active substance: L-threo-3,4-dihydroxyphenylserine
Designated 2007-08-02. Orphan designation status: Withdrawn.
First published: 2009-09-21. Last updated: 2022-01-28.

Intended use: Treatment of orthostatic hypotension in patients with multiple system atrophy

Source: https://www.ema.europa.eu/en/medicines/human/orphan-designations/eu-3-07-466